EMA orphan designation EU/3/16/1825
Active substance: recombinant adeno-associated viral vector serotype 9 containing the human N-alpha-acetylglucosaminidase gene
Designated 2017-01-12. Orphan designation status: Positive.
First published: 2017-07-14. Last updated: 2024-10-23.

Intended use: Treatment of mucopolysaccharidosis type IIIB (Sanfilippo B syndrome)

Source: https://www.ema.europa.eu/en/medicines/human/orphan-designations/eu-3-16-1825